EMA orphan designation EU/3/16/1636
Active substance: sindbis virus envelope pseudotyped lentiviral vector encoding New York oesophageal squamous cell carcinoma-1 protein
Designated 2016-03-21. Orphan designation status: Withdrawn.
First published: 2016-05-04. Last updated: 2019-10-15.

Intended use: Treatment of soft tissue sarcoma

Source: https://www.ema.europa.eu/en/medicines/human/orphan-designations/eu-3-16-1636